EMA orphan designation EU/3/16/1630
Active substance: Fenretinide
Designated 2016-03-21. Orphan designation status: Withdrawn.
First published: 2016-05-03. Last updated: 2022-01-19.

Intended use: Treatment of cutaneous T-cell lymphoma

Source: https://www.ema.europa.eu/en/medicines/human/orphan-designations/eu-3-16-1630